EMA orphan designation EU/3/16/1704
Active substance: sirolimus
Designated 2016-07-14. Orphan designation status: Positive.
First published: 2016-09-06. Last updated: 2022-02-18.

Intended use: Treatment of sporadic lymphangioleiomyomatosis

Source: https://www.ema.europa.eu/en/medicines/human/orphan-designations/eu-3-16-1704